EMA orphan designation EU/3/10/813
Active substance: recombinant human arylsulfatase A
Designated 2010-11-26. Orphan designation status: Positive.
First published: 2010-12-17. Last updated: 2022-10-04.

Intended use: treatment of metachromatic leukodystrophy

Source: https://www.ema.europa.eu/en/medicines/human/orphan-designations/eu-3-10-813